EMA orphan designation EU/3/09/687
Active substance: patupilone
Designated 2009-11-05. Orphan designation status: Withdrawn.
First published: 2009-11-18. Last updated: 2015-02-11.

Intended use: Treatment of primary peritoneal cancer

Source: https://www.ema.europa.eu/en/medicines/human/orphan-designations/eu-3-09-687